EMA orphan designation EU/3/22/2632
Active substance: Lithium carbonate
Designated 2022-06-21. Orphan designation status: Positive.
First published: 2022-09-22.

Intended use: treatment of TBR1-related disorder

Source: https://www.ema.europa.eu/en/medicines/human/orphan-designations/eu-3-22-2632